EMA orphan designation EU/3/10/757
Active substance: Pomalidomide
Designated 2010-07-27. Orphan designation status: Withdrawn.
First published: 2010-08-06. Last updated: 2017-02-10.

Intended use: Treatment of primary myelofibrosis

Source: https://www.ema.europa.eu/en/medicines/human/orphan-designations/eu-3-10-757